EMA orphan designation EU/3/23/2888
Active substance: recombinant human heparan-N-sulfatase
Designated 2024-01-12. Orphan designation status: Positive.
First published: 2024-04-11.

Intended use: Treatment of mucopolysaccharidosis type IIIA (Sanfilippo A syndrome)

Source: https://www.ema.europa.eu/en/medicines/human/orphan-designations/eu-3-23-2888